EMA orphan designation EU/3/09/653
Active substance: Eculizumab
Designated 2009-07-24. Orphan designation status: Positive.
First published: 2009-09-11. Last updated: 2019-11-20.

Intended use: Treatment of atypical haemolytic uraemic syndrome (aHUS)

Source: https://www.ema.europa.eu/en/medicines/human/orphan-designations/eu-3-09-653